EMA orphan designation EU/3/24/3016
Active substance: Elraglusib
Designated 2025-01-16. Orphan designation status: Positive.
First published: 2025-02-20.

Intended use: Treatment of soft tissue sarcoma

Source: https://www.ema.europa.eu/en/medicines/human/orphan-designations/eu-3-24-3016